EMA orphan designation EU/3/15/1500: Hepcludex
Designated 2015-06-19. Orphan designation status: Positive. EMA product number: EMEA/H/C/004854.
First published: 2015-07-27. Last updated: 2021-06-30.

Intended use: Treatment of hepatitis delta virus infection

Source: https://www.ema.europa.eu/en/medicines/human/orphan-designations/eu-3-15-1500